EMA orphan designation EU/3/16/1681
Active substance: Humanised monoclonal antibody targeting interleukin-15
Designated 2016-06-27. Orphan designation status: Positive.
First published: 2016-07-26. Last updated: 2024-10-22.

Intended use: Treatment of eosinophilic oesophagitis

Source: https://www.ema.europa.eu/en/medicines/human/orphan-designations/eu-3-16-1681